EMA orphan designation EU/3/09/622
Active substance: N-(5-tert-Butylisoxazol-3-yl)-N'-{4-[7-(2-(morpholin-4-yl)ethoxy) imidazo[2,1-b][1,3]benzothiazol-2-yl]phenyl}urea di-hydrochloride salt
Designated 2009-03-23. Orphan designation status: Withdrawn.
First published: 2009-04-08. Last updated: 2026-05-26.

Intended use: Treatment of acute myeloid leukaemia

Source: https://www.ema.europa.eu/en/medicines/human/orphan-designations/eu-3-09-622